EMA orphan designation EU/3/22/2618
Active substance: Icerguastat acetate
Designated 2022-05-16. Orphan designation status: Positive.
First published: 2022-12-14.

Intended use: Treatment of amyotrophic lateral sclerosis

Source: https://www.ema.europa.eu/en/medicines/human/orphan-designations/eu-3-22-2618